EMA orphan designation EU/3/18/2017
Active substance: ambroxol hydrochloride
Designated 2018-05-25. Orphan designation status: Positive.
First published: 2018-07-24. Last updated: 2018-07-24.

Intended use: Treatment of amyotrophic lateral sclerosis

Source: https://www.ema.europa.eu/en/medicines/human/orphan-designations/eu-3-18-2017